EMA orphan designation EU/3/22/2629
Active substance: 4-[[(3S)-1-benzylpyrrolidin-3-yl]-methylamino]-2-fluoro-5-methyl-N-(1,3-thiazol-4-yl)benzenesulfonamide
Designated 2022-06-21. Orphan designation status: Positive.
First published: 2022-09-15.

Intended use: Treatment of SCN8A developmental and epileptic encephalopathy

Source: https://www.ema.europa.eu/en/medicines/human/orphan-designations/eu-3-22-2629